EMA orphan designation EU/3/00/013: Ethyl Eicosapent soft gelatin capsules
Active substance: Ethyl Eicosapentaenoate
Designated 2000-12-29. Orphan designation status: Positive. EMA product number: EMEA/H/C/001148.
First published: 2006-01-04. Last updated: 2006-01-04.

Intended use: Treatment of Huntington’s disease

Source: https://www.ema.europa.eu/en/medicines/human/orphan-designations/eu-3-00-013